EMA orphan designation EU/3/20/2268
Active substance: florbetaben (18F)
Designated 2020-04-22. Orphan designation status: Positive.
First published: 2020-08-12.

Intended use: Diagnosis of AL amyloidosis

Source: https://www.ema.europa.eu/en/medicines/human/orphan-designations/eu-3-20-2268